EMA orphan designation EU/3/16/1676
Active substance: Cyclocreatine
Designated 2016-06-27. Orphan designation status: Withdrawn.
First published: 2016-07-26. Last updated: 2019-07-09.

Intended use: Treatment of creatine deficiency syndromes

Source: https://www.ema.europa.eu/en/medicines/human/orphan-designations/eu-3-16-1676